EMA orphan designation EU/3/22/2682
Active substance: vutrisiran
Designated 2022-08-10. Orphan designation status: Withdrawn.
First published: 2022-12-15. Last updated: 2026-05-26.

Intended use: Treatment of Stargardt's disease

Source: https://www.ema.europa.eu/en/medicines/human/orphan-designations/eu-3-22-2682